EMA orphan designation EU/3/11/909
Active substance: macitentan
Designated 2011-09-27. Orphan designation status: Withdrawn.
First published: 2011-10-17. Last updated: 2026-05-26.

Intended use: Treatment of pulmonary arterial hypertension

Source: https://www.ema.europa.eu/en/medicines/human/orphan-designations/eu-3-11-909